EMA orphan designation EU/3/18/2045
Active substance: ex-vivo fused autologous human bone marrow-derived mesenchymal stem cell with allogenic human myoblast
Designated 2018-07-31. Orphan designation status: Positive.
First published: 2018-10-10. Last updated: 2023-04-28.

Intended use: Treatment of Duchenne muscular dystrophy

Source: https://www.ema.europa.eu/en/medicines/human/orphan-designations/eu-3-18-2045